EMA orphan designation EU/3/12/1038
Active substance: recombinant anti-CD3-bi-single-chain-Fv-diphtheria toxin fusion protein
Designated 2012-08-09. Orphan designation status: Positive.
First published: 2012-09-27. Last updated: 2022-02-04.

Intended use: Treatment of peripheral T-cell lymphoma (nodal, other extranodal and leukaemic/disseminated)

Source: https://www.ema.europa.eu/en/medicines/human/orphan-designations/eu-3-12-1038